EMA orphan designation EU/3/22/2738
Active substance: octreotide acetate
Designated 2023-01-13. Orphan designation status: Withdrawn.
First published: 2023-03-23.

Intended use: Treatment of carcinoid syndrome

Source: https://www.ema.europa.eu/en/medicines/human/orphan-designations/eu-3-22-2738